EMA orphan designation EU/3/19/2186
Active substance: pevonedistat
Designated 2019-07-25. Orphan designation status: Withdrawn.
First published: 2019-09-24. Last updated: 2022-02-18.

Intended use: Treatment of acute myeloid leukaemia

Source: https://www.ema.europa.eu/en/medicines/human/orphan-designations/eu-3-19-2186